EMA orphan designation EU/3/10/762
Active substance: Bosutinib
Designated 2010-08-04. Orphan designation status: Withdrawn.
First published: 2010-08-11. Last updated: 2018-05-07.

Intended use: Treatment of chronic myeloid leukaemia

Source: https://www.ema.europa.eu/en/medicines/human/orphan-designations/eu-3-10-762